EMA orphan designation EU/3/17/1832
Active substance: alpha-tocopherol; ascorbic acid
Designated 2017-02-27. Orphan designation status: Positive.
First published: 2017-03-31. Last updated: 2017-03-31.

Intended use: Treatment of fragile X syndrome

Source: https://www.ema.europa.eu/en/medicines/human/orphan-designations/eu-3-17-1832